EMA orphan designation EU/3/25/3162
Active substance: domvanalimab
Designated 2025-12-09. Orphan designation status: Positive.
First published: 2026-03-27.

Intended use: Treatment of gastric cancer

Source: https://www.ema.europa.eu/en/medicines/human/orphan-designations/eu-3-25-3162